EMA orphan designation EU/3/03/160
Active substance: antisense oligonucleotide (TATCCGGAGGGCTCGCCATGCTGCT)
Designated 2003-10-02. Orphan designation status: Positive.
First published: 2004-04-26. Last updated: 2004-04-26.

Intended use: Treatment of retinopathy of prematurity

Source: https://www.ema.europa.eu/en/medicines/human/orphan-designations/eu-3-03-160